EMA orphan designation EU/3/10/842
Active substance: nimorazole
Designated 2011-02-23. Orphan designation status: Withdrawn.
First published: 2011-03-04. Last updated: 2026-05-18.

Intended use: Treatment of squamous-cell carcinoma of the head and neck in patients undergoing radiotherapy

Source: https://www.ema.europa.eu/en/medicines/human/orphan-designations/eu-3-10-842